EMA orphan designation EU/3/11/876
Active substance: viral vector containing DNA encoding the human SMN protein
Designated 2011-06-21. Orphan designation status: Positive.
First published: 2011-07-12. Last updated: 2011-07-12.

Intended use: Treatment of 5q spinal muscular atrophy

Source: https://www.ema.europa.eu/en/medicines/human/orphan-designations/eu-3-11-876